EMA orphan designation EU/3/07/454
Active substance: recombinant adeno-associated viral vector containing human acid alfa-glucosidase-gene
Designated 2007-07-09. Orphan designation status: Withdrawn.
First published: 2007-12-04. Last updated: 2011-04-07.

Intended use: Treatment of glycogen storage disease type II (Pompe's disease)

Source: https://www.ema.europa.eu/en/medicines/human/orphan-designations/eu-3-07-454